EMA orphan designation EU/3/20/2260
Active substance: allogeneic multi-virus specific T lymphocytes targeting BK virus, cytomegalovirus, human herpesvirus-6, Epstein Barr virus and adenovirus
Designated 2020-03-24. Orphan designation status: Positive.
First published: 2020-08-12. Last updated: 2023-06-08.

Intended use: Treatment in haematopoietic stem cell transplantation

Source: https://www.ema.europa.eu/en/medicines/human/orphan-designations/eu-3-20-2260